EMA orphan designation EU/3/14/1296
Active substance: adeno-associated viral vector serotype 9 containing the human cardiac calsequestrin gene
Designated 2014-07-29. Orphan designation status: Positive.
First published: 2014-09-01. Last updated: 2021-04-09.

Intended use: Treatment of catecholaminergic polymorphic ventricular tachycardia

Source: https://www.ema.europa.eu/en/medicines/human/orphan-designations/eu-3-14-1296